EMA orphan designation EU/3/20/2326
Active substance: autologous CD34+ cells transduced with a lentiviral vector encoding glucosylceramidase beta
Designated 2020-08-21. Orphan designation status: Withdrawn.
First published: 2020-11-25. Last updated: 2024-04-09.

Intended use: Treatment of Gaucher disease

Source: https://www.ema.europa.eu/en/medicines/human/orphan-designations/eu-3-20-2326